EMA orphan designation EU/3/16/1788: Prohippur
Active substance: sodium benzoate
Designated 2016-11-18. Orphan designation status: Positive. EMA product number: EMEA/H/C/004150.
First published: 2016-12-14. Last updated: 2016-12-14.

Intended use: Treatment of N-acetylglutamate synthetase (NAGS) deficiency

Source: https://www.ema.europa.eu/en/medicines/human/orphan-designations/eu-3-16-1788